EMA orphan designation EU/3/17/1833
Active substance: autologous T-cells transduced with lentiviral vector encoding an anti-SLAMF7 CD28/CD3-zeta chimeric antigen receptor
Designated 2017-02-27. Orphan designation status: Positive.
First published: 2017-03-31. Last updated: 2017-03-31.

Intended use: Treatment of plasma cell myeloma

Source: https://www.ema.europa.eu/en/medicines/human/orphan-designations/eu-3-17-1833